EMA orphan designation EU/3/25/3054
Active substance: taladegib
Designated 2025-05-22. Orphan designation status: Positive.
First published: 2025-06-30.

Intended use: Treatment of idiopathic pulmonary fibrosis

Source: https://www.ema.europa.eu/en/medicines/human/orphan-designations/eu-3-25-3054